EMA orphan designation EU/3/14/1279
Active substance: 4-(4-Methoxy-phenylamino)-6-methylcarbamyl-quinoline-3-carboxylic acid
Designated 2014-06-04. Orphan designation status: Positive.
First published: 2014-07-10. Last updated: 2014-07-10.

Intended use: Prevention of scarring post glaucoma filtration surgery

Source: https://www.ema.europa.eu/en/medicines/human/orphan-designations/eu-3-14-1279